EMA orphan designation EU/3/18/2074: Ayvakyt
Active substance: avapritinib
Designated 2018-10-26. Orphan designation status: Positive. EMA product number: EMEA/H/C/005208.
First published: 2019-01-04. Last updated: 2022-05-18.

Intended use: Treatment of mastocytosis

Source: https://www.ema.europa.eu/en/medicines/human/orphan-designations/eu-3-18-2074